EMA orphan designation EU/3/22/2711
Active substance: copper nanocluster conjugated to acetate, histidinate and ascorbate
Designated 2022-11-11. Orphan designation status: Positive.
First published: 2023-01-23.

Intended use: Treatment of Menkes disease

Source: https://www.ema.europa.eu/en/medicines/human/orphan-designations/eu-3-22-2711